EMA orphan designation EU/3/17/1864
Active substance: emeramide
Designated 2017-04-20. Orphan designation status: Positive.
First published: 2017-05-15. Last updated: 2017-08-16.

Intended use: Prevention of mercury toxicity

Source: https://www.ema.europa.eu/en/medicines/human/orphan-designations/eu-3-17-1864